EMA orphan designation EU/3/02/112
Active substance: Doxorubicin carbon; iron magnetically targeted microparticles
Designated 2002-09-11. Orphan designation status: Withdrawn.
First published: 2009-10-15. Last updated: 2009-10-15.

Intended use: Treatment of hepatocellular carcinoma

Source: https://www.ema.europa.eu/en/medicines/human/orphan-designations/eu-3-02-112